EMA orphan designation EU/3/24/2987
Active substance: 3-(5-(2-Hydroxy-2-methylpropoxy)-6-methylpyrazin-2-yl)-1H-indole-7-carbonitrile
Designated 2024-11-10. Orphan designation status: Positive.
First published: 2024-11-20.

Intended use: Treatment of X-linked spinal and bulbar muscular atrophy (Kennedy's disease)

Source: https://www.ema.europa.eu/en/medicines/human/orphan-designations/eu-3-24-2987